EMA orphan designation EU/3/14/1418
Active substance: A lentiviral vector pseudotyped by the New-Jersey serotype of the vesicular stomatitis virus G protein encoding an antigen derived from the Tax, HBZ, p12I and p30II HTLV-1 proteins
Designated 2015-01-15. Orphan designation status: Positive.
First published: 2015-03-05. Last updated: 2015-03-05.

Intended use: Treatment of adult T-cell leukaemia/lymphoma

Source: https://www.ema.europa.eu/en/medicines/human/orphan-designations/eu-3-14-1418